EMA orphan designation EU/3/04/238
Active substance: recombinant human insulin-like growth factor-I; recombinant human insulin-like growth factor binding protein-3
Designated 2004-10-21. Orphan designation status: Withdrawn.
First published: 2009-02-20. Last updated: 2011-06-21.

Intended use: Treatment of leprechaunism

Source: https://www.ema.europa.eu/en/medicines/human/orphan-designations/eu-3-04-238